EMA orphan designation EU/3/16/1631
Active substance: Florilglutamic acid (18F)
Designated 2016-03-21. Orphan designation status: Withdrawn.
First published: 2016-05-02. Last updated: 2024-04-09.

Intended use: Diagnosis of glioma

Source: https://www.ema.europa.eu/en/medicines/human/orphan-designations/eu-3-16-1631